EMA orphan designation EU/3/12/1017
Active substance: talarozole
Designated 2012-07-04. Orphan designation status: Withdrawn.
First published: 2012-07-24. Last updated: 2016-01-19.

Intended use: Treatment of recessive X-linked ichthyosis

Source: https://www.ema.europa.eu/en/medicines/human/orphan-designations/eu-3-12-1017